EMA orphan designation EU/3/23/2807
Active substance: paclitaxel obaluronate
Designated 2023-07-25. Orphan designation status: Positive.
First published: 2024-02-01.

Intended use: Treatment of malignant mesothelioma

Source: https://www.ema.europa.eu/en/medicines/human/orphan-designations/eu-3-23-2807